EMA orphan designation EU/3/18/2068: Sogroya
Active substance: somapacitan
Designated 2018-08-24. Orphan designation status: Positive. EMA product number: EMEA/H/C/005030.
First published: 2018-12-19. Last updated: 2021-04-15.

Intended use: Treatment of growth hormone deficiency

Source: https://www.ema.europa.eu/en/medicines/human/orphan-designations/eu-3-18-2068